EMA orphan designation EU/3/19/2191
Active substance: relacorilant
Designated 2019-08-21. Orphan designation status: Positive.
First published: 2020-01-14. Last updated: 2024-10-22.

Intended use: Treatment of pancreatic cancer

Source: https://www.ema.europa.eu/en/medicines/human/orphan-designations/eu-3-19-2191